EMA orphan designation EU/3/21/2456
Active substance: adeno-associated virus serotype 9 containing the human FXN gene isoform 1
Designated 2021-06-21. Orphan designation status: Withdrawn.
First published: 2022-05-11.

Intended use: Treatment of Friedreich's ataxia

Source: https://www.ema.europa.eu/en/medicines/human/orphan-designations/eu-3-21-2456